EMA orphan designation EU/3/16/1693
Active substance: adeno-associated viral vector serotype 2.7m8 containing the ChrimsonR-tdTomato gene
Designated 2016-07-14. Orphan designation status: Positive.
First published: 2016-09-06. Last updated: 2016-09-06.

Intended use: Treatment of retinitis pigmentosa

Source: https://www.ema.europa.eu/en/medicines/human/orphan-designations/eu-3-16-1693